EMA orphan designation EU/3/11/905
Active substance: Kifunensine
Designated 2011-09-27. Orphan designation status: Withdrawn.
First published: 2011-10-17. Last updated: 2014-06-19.

Intended use: Treatment of alpha-sarcoglycanopathy

Source: https://www.ema.europa.eu/en/medicines/human/orphan-designations/eu-3-11-905